EMA orphan designation EU/3/22/2742
Active substance: valganciclovir; nanatinostat
Designated 2023-01-13. Orphan designation status: Withdrawn.
First published: 2023-03-23. Last updated: 2025-09-04.

Intended use: Treatment of diffuse large B-cell lymphoma

Source: https://www.ema.europa.eu/en/medicines/human/orphan-designations/eu-3-22-2742